EMA orphan designation EU/3/14/1302
Active substance: Oxytocin
Designated 2014-07-29. Orphan designation status: Positive.
First published: 2014-09-01. Last updated: 2021-01-25.

Intended use: Treatment of Prader-Willi syndrome

Source: https://www.ema.europa.eu/en/medicines/human/orphan-designations/eu-3-14-1302